EMA orphan designation EU/3/12/1014
Active substance: talarozole
Designated 2012-07-04. Orphan designation status: Withdrawn.
First published: 2012-07-24. Last updated: 2016-01-19.

Intended use: Treatment of keratinopathic ichthyosis

Source: https://www.ema.europa.eu/en/medicines/human/orphan-designations/eu-3-12-1014